EMA orphan designation EU/3/06/356
Active substance: 4-Amino-5-oxo-4 (pyridinium-1-ylmethyl) proline
Designated 2006-02-16. Orphan designation status: Positive.
First published: 2006-08-24. Last updated: 2011-04-04.

Intended use: Treatment of renal-cell carcinoma

Source: https://www.ema.europa.eu/en/medicines/human/orphan-designations/eu-3-06-356